EMA orphan designation EU/3/25/3119
Active substance: zilurgisertib
Designated 2025-08-22. Orphan designation status: Positive.
First published: 2026-04-10.

Intended use: Treatment of fibrodysplasia ossificans progressiva

Source: https://www.ema.europa.eu/en/medicines/human/orphan-designations/eu-3-25-3119